EMA orphan designation EU/3/01/055
Active substance: cladribine
Designated 2001-09-18. Orphan designation status: Expired.
First published: 2005-11-29. Last updated: 2014-06-18.

Intended use: Treatment of indolent non-Hodgkin's lymphoma

Source: https://www.ema.europa.eu/en/medicines/human/orphan-designations/eu-3-01-055